EMA orphan designation EU/3/16/1755
Active substance: melatonin
Designated 2016-10-14. Orphan designation status: Positive.
First published: 2016-11-15. Last updated: 2018-10-23.

Intended use: Treatment of Smith-Magenis syndrome

Source: https://www.ema.europa.eu/en/medicines/human/orphan-designations/eu-3-16-1755